EMA orphan designation EU/3/24/2915
Active substance: serdexmethylphenidate
Designated 2024-05-24. Orphan designation status: Positive.
First published: 2024-08-22.

Intended use: Treatment of idiopathic hypersomnia

Source: https://www.ema.europa.eu/en/medicines/human/orphan-designations/eu-3-24-2915